EMA orphan designation EU/3/24/2932
Active substance: topiramate
Designated 2024-06-28. Orphan designation status: Positive.
First published: 2024-08-22.

Intended use: Treatment of neonatal encephalopathy

Source: https://www.ema.europa.eu/en/medicines/human/orphan-designations/eu-3-24-2932